EMA orphan designation EU/3/08/598
Active substance: exon 44 specific phosphorothioate oligonucleotide
Designated 2009-02-27. Orphan designation status: Positive.
First published: 2009-06-29. Last updated: 2016-12-02.

Intended use: Treatment of Duchenne muscular dystrophy

Source: https://www.ema.europa.eu/en/medicines/human/orphan-designations/eu-3-08-598